EMA orphan designation EU/3/13/1185: Soliris
Active substance: Eculizumab
Designated 2013-08-05. Orphan designation status: Positive. EMA product number: EMEA/H/C/000791.
First published: 2013-09-16. Last updated: 2019-11-20.

Intended use: Treatment of neuromyelitis optica spectrum disorders

Source: https://www.ema.europa.eu/en/medicines/human/orphan-designations/eu-3-13-1185